EMA orphan designation EU/3/16/1750
Active substance: Exendin (9-39)
Designated 2016-10-14. Orphan designation status: Positive.
First published: 2016-11-14. Last updated: 2019-08-19.

Intended use: Treatment of noninsulinoma pancreatogenous hypoglycaemia syndrome

Source: https://www.ema.europa.eu/en/medicines/human/orphan-designations/eu-3-16-1750